EMA orphan designation EU/3/08/599
Active substance: exon 51 specific phosphorothioate oligonucleotide
Designated 2009-02-27. Orphan designation status: Positive.
First published: 2009-06-29. Last updated: 2015-05-27.

Intended use: Treatment of Duchenne muscular dystrophy

Source: https://www.ema.europa.eu/en/medicines/human/orphan-designations/eu-3-08-599